EMA orphan designation EU/3/09/656
Active substance: recombinant histidine-tagged idiotype immunoglobulin Fab fragment of clonal B-cell receptors
Designated 2009-07-24. Orphan designation status: Positive.
First published: 2009-09-11. Last updated: 2011-05-31.

Intended use: Treatment of diffuse large B-cell lymphoma

Source: https://www.ema.europa.eu/en/medicines/human/orphan-designations/eu-3-09-656